EMA orphan designation EU/3/21/2428
Active substance: macitentan
Designated 2021-05-20. Orphan designation status: Withdrawn.
First published: 2022-03-16. Last updated: 2026-05-26.

Intended use: Treatment of functional single ventricle congenital heart disease

Source: https://www.ema.europa.eu/en/medicines/human/orphan-designations/eu-3-21-2428